EMA orphan designation EU/3/13/1151
Active substance: belinostat
Designated 2013-07-17. Orphan designation status: Positive.
First published: 2013-08-21. Last updated: 2016-02-08.

Intended use: Treatment of malignant thymoma

Source: https://www.ema.europa.eu/en/medicines/human/orphan-designations/eu-3-13-1151